EMA orphan designation EU/3/21/2563
Active substance: olorofim
Designated 2022-01-14. Orphan designation status: Positive.
First published: 2022-06-09. Last updated: 2024-04-08.

Intended use: Treatment of invasive Scopulariopsis

Source: https://www.ema.europa.eu/en/medicines/human/orphan-designations/eu-3-21-2563